EMA orphan designation EU/3/17/1830
Active substance: 5-(4,6-dimorpholino-1,3,5-triazin-2-yl)-4-(trifluoromethyl)pyridin-2-amine (bimiralisib)
Designated 2017-02-27. Orphan designation status: Positive.
First published: 2017-03-31. Last updated: 2021-06-10.

Intended use: Treatment of diffuse large B-cell lymphoma

Source: https://www.ema.europa.eu/en/medicines/human/orphan-designations/eu-3-17-1830